EMA orphan designation EU/3/18/2090
Active substance: fidanacogene elaparvovec
Designated 2018-11-19. Orphan designation status: Withdrawn.
First published: 2019-02-19. Last updated: 2024-04-09.

Intended use: Treatment of haemophilia B

Source: https://www.ema.europa.eu/en/medicines/human/orphan-designations/eu-3-18-2090